EMA orphan designation EU/3/07/503
Active substance: recombinant human hepatitis C monoclonal antibody against C4 region of E1
Designated 2007-12-06. Orphan designation status: Positive.
First published: 2008-08-18. Last updated: 2008-08-18.

Intended use: Prevention of recurrent hepatitis C virus induced liver disease in liver transplant recipients

Source: https://www.ema.europa.eu/en/medicines/human/orphan-designations/eu-3-07-503